EMA orphan designation EU/3/19/2172
Active substance: Gaboxadol monohydrate
Designated 2019-06-28. Orphan designation status: Positive.
First published: 2019-10-07.

Intended use: Treatment of Angelman syndrome

Source: https://www.ema.europa.eu/en/medicines/human/orphan-designations/eu-3-19-2172